EMA orphan designation EU/3/24/2975
Active substance: (E)-2-((4-((4-Benzyl(ethyl)amino)phenyl)diazinyl)phenyl)amino-N,N,N-triethyl-2-oxoethan-1-aminium chloride
Designated 2024-08-21. Orphan designation status: Positive.
First published: 2024-09-19.

Intended use: Treatment of syndromic inherited retinal dystrophies of the rod-dominant phenotype

Source: https://www.ema.europa.eu/en/medicines/human/orphan-designations/eu-3-24-2975